EMA orphan designation EU/3/19/2201
Active substance: Poly(oxy-1,2-ethanediyl), alpha-hydro-omega-hydroxy-,15,15'-diester with N-acetyl-L-isoleucyl-L-cysteinyl-L-valyl-1-methyl-L-tryptophyl-L-glutaminyl-L-alpha-aspartyl-L-tryptophylglycyl-L-alanyl-L-histidyl-L-arginyl-L-cysteinyl-L-threonyl-2-[2-(2-aminoethoxy)ethoxy]acetyl-N6-carboxy-L-lysinamide cyclic (2.fwdarw.12)-(disulfide); where two identical synthetic peptide domains are covalently linked at the ends of the polyethylene glycol chain (pegcetacoplan)
Designated 2019-08-21. Orphan designation status: Positive.
First published: 2020-01-16. Last updated: 2021-02-03.

Intended use: Treatment of C3 glomerulopathy

Source: https://www.ema.europa.eu/en/medicines/human/orphan-designations/eu-3-19-2201